EMA orphan designation EU/3/05/310: Orepaxam
Active substance: treprostinil diethanolamine
Designated 2005-08-26. Orphan designation status: Positive. EMA product number: EMEA/H/C/005990.
First published: 2009-09-23. Last updated: 2022-01-13.

Intended use: Treatment of pulmonary arterial hypertension and chronic thromboembolic pulmonary hypertension

Source: https://www.ema.europa.eu/en/medicines/human/orphan-designations/eu-3-05-310